EMA orphan designation EU/3/22/2654
Active substance: (S)-1-(4-(1-(3,4,5-trimethoxyphenyl)-1H-imidazol-4-ylamino)thieno[2,3-d]pyrimidin-2-yl)pyrrolidine-2-carboxamide
Designated 2022-07-18. Orphan designation status: Withdrawn.
First published: 2023-01-23. Last updated: 2026-05-18.

Intended use: Treatment of fibrodysplasia ossificans progressiva

Source: https://www.ema.europa.eu/en/medicines/human/orphan-designations/eu-3-22-2654